EMA orphan designation EU/3/10/777: Nulibry
Active substance: cyclic pyranopterin monophosphate (fosdenopterin)
Designated 2010-09-20. Orphan designation status: Positive. EMA product number: EMEA/H/C/005378.
First published: 2010-10-18. Last updated: 2023-07-17.

Intended use: Treatment of molybdenum-cofactor deficiency type A

Source: https://www.ema.europa.eu/en/medicines/human/orphan-designations/eu-3-10-777